EMA orphan designation EU/3/09/712
Active substance: recombinant kallikrein inhibitor
Designated 2010-01-29. Orphan designation status: Positive.
First published: 2010-02-25. Last updated: 2012-02-09.

Intended use: Treatment of Netherton syndrome

Source: https://www.ema.europa.eu/en/medicines/human/orphan-designations/eu-3-09-712